EMA orphan designation EU/3/06/380
Active substance: Diphenylcyclopropenone
Designated 2006-06-29. Orphan designation status: Withdrawn.
First published: 2009-04-24. Last updated: 2013-09-23.

Intended use: Treatment of alopecia universalis

Source: https://www.ema.europa.eu/en/medicines/human/orphan-designations/eu-3-06-380